EMA orphan designation EU/3/14/1381
Active substance: adeno-associated viral vector serotype 8 containing the human MD1 gene
Designated 2014-11-19. Orphan designation status: Positive.
First published: 2015-01-16. Last updated: 2015-01-16.

Intended use: Treatment of Duchenne muscular dystrophy

Source: https://www.ema.europa.eu/en/medicines/human/orphan-designations/eu-3-14-1381